EMA orphan designation EU/3/15/1525
Active substance: triheptanoin
Designated 2015-07-28. Orphan designation status: Positive.
First published: 2015-12-18. Last updated: 2020-11-11.

Intended use: Treatment of mitochondrial trifunctional protein deficiency

Source: https://www.ema.europa.eu/en/medicines/human/orphan-designations/eu-3-15-1525